EMA orphan designation EU/3/06/370
Active substance: decitabine
Designated 2006-06-08. Orphan designation status: Positive.
First published: 2007-07-19. Last updated: 2013-09-19.

Intended use: Treatment of acute myeloid leukaemia

Source: https://www.ema.europa.eu/en/medicines/human/orphan-designations/eu-3-06-370